EMA orphan designation EU/3/09/646
Active substance: talampanel
Designated 2009-06-12. Orphan designation status: Withdrawn.
First published: 2009-07-01. Last updated: 2010-11-18.

Intended use: Treatment of amyotrophic lateral sclerosis

Source: https://www.ema.europa.eu/en/medicines/human/orphan-designations/eu-3-09-646